EMA orphan designation EU/3/22/2744
Active substance: Efzofitimod
Designated 2023-01-13. Orphan designation status: Positive.
First published: 2023-03-23.

Intended use: Treatment of sarcoidosis

Source: https://www.ema.europa.eu/en/medicines/human/orphan-designations/eu-3-22-2744